EMA orphan designation EU/3/14/1341: Dropcys
Active substance: cysteamine hydrochloride
Designated 2014-10-15. Orphan designation status: Positive. EMA product number: EMEA/H/C/004038.
First published: 2014-11-12. Last updated: 2014-11-12.

Intended use: Treatment of cystinosis

Source: https://www.ema.europa.eu/en/medicines/human/orphan-designations/eu-3-14-1341